EMA orphan designation EU/3/18/2109
Active substance: adeno-associated virus serotype HSC15 expressing human phenylalanine hydroxylase
Designated 2018-12-14. Orphan designation status: Withdrawn.
First published: 2019-03-08. Last updated: 2023-08-08.

Intended use: Treatment of phenylalanine hydroxylase deficiency

Source: https://www.ema.europa.eu/en/medicines/human/orphan-designations/eu-3-18-2109